EMA orphan designation EU/3/08/538
Active substance: amrubicin hydrochloride
Designated 2008-04-02. Orphan designation status: Withdrawn.
First published: 2009-09-17. Last updated: 2016-08-11.

Intended use: Treatment of small cell lung cancer

Source: https://www.ema.europa.eu/en/medicines/human/orphan-designations/eu-3-08-538